EMA orphan designation EU/3/13/1144
Active substance: recombinant human alpha-N-acetylglucosaminidase
Designated 2013-06-19. Orphan designation status: Withdrawn.
First published: 2013-07-11. Last updated: 2017-10-19.

Intended use: Treatment of mucopolysaccharidosis type IIIB (Sanfilippo B syndrome)

Source: https://www.ema.europa.eu/en/medicines/human/orphan-designations/eu-3-13-1144